EMA orphan designation EU/3/26/3201
Active substance: Measles virus Schwarz strain (live, attenuated)
Designated 2026-02-23. Orphan designation status: Positive.
First published: 2026-04-10.

Intended use: Treatment of mesothelioma

Source: https://www.ema.europa.eu/en/medicines/human/orphan-designations/eu-3-26-3201